EMA orphan designation EU/3/23/2750
Active substance: Fosmanogepix
Designated 2023-02-15. Orphan designation status: Positive.
First published: 2024-01-25. Last updated: 2024-10-02.

Intended use: Treatment of lomentosporiosis

Source: https://www.ema.europa.eu/en/medicines/human/orphan-designations/eu-3-23-2750